EMA orphan designation EU/3/16/1779
Active substance: human monoclonal antibody against activin A
Designated 2016-11-18. Orphan designation status: Positive.
First published: 2016-12-14. Last updated: 2021-10-07.

Intended use: Treatment of fibrodysplasia ossificans progressiva

Source: https://www.ema.europa.eu/en/medicines/human/orphan-designations/eu-3-16-1779